EMA orphan designation EU/3/23/2876: Imdylltra
Active substance: tarlatamab
Designated 2024-01-12. Orphan designation status: Positive. EMA product number: EMEA/H/C/006451.
First published: 2024-04-11. Last updated: 2026-03-27.

Intended use: Treatment of small cell lung cancer

Source: https://www.ema.europa.eu/en/medicines/human/orphan-designations/eu-3-23-2876